EMA orphan designation EU/3/16/1768
Active substance: xenon
Designated 2016-10-14. Orphan designation status: Positive.
First published: 2016-11-15. Last updated: 2016-11-15.

Intended use: Treatment of ischaemia/reperfusion injury associated with cardiac arrest

Source: https://www.ema.europa.eu/en/medicines/human/orphan-designations/eu-3-16-1768